EMA orphan designation EU/3/24/2973: Tryngolza
Active substance: olezarsen sodium
Designated 2024-08-21. Orphan designation status: Positive. EMA product number: EMEA/H/C/006477.
First published: 2024-09-19.

Intended use: Treatment of familial chylomicronaemia syndrome

Source: https://www.ema.europa.eu/en/medicines/human/orphan-designations/eu-3-24-2973